EMA orphan designation EU/3/13/1133: Livtencity
Active substance: Maribavir
Designated 2013-06-07. Orphan designation status: Positive. EMA product number: EMEA/H/C/005787.
First published: 2013-06-25. Last updated: 2022-11-24.

Intended use: Treatment of cytomegalovirus disease in patients with impaired cell-mediated immunity

Source: https://www.ema.europa.eu/en/medicines/human/orphan-designations/eu-3-13-1133